EMA orphan designation EU/3/16/1744
Active substance: autologous mononuclear cells derived from human cord blood
Designated 2016-10-14. Orphan designation status: Positive.
First published: 2016-11-14. Last updated: 2016-11-14.

Intended use: Treatment of periventricular leukomalacia

Source: https://www.ema.europa.eu/en/medicines/human/orphan-designations/eu-3-16-1744